EMA orphan designation EU/3/16/1684
Active substance: modified mRNA encoding the UGT1A1 protein
Designated 2016-06-27. Orphan designation status: Positive.
First published: 2016-07-26. Last updated: 2022-09-14.

Intended use: Treatment of Crigler-Najjar syndrome

Source: https://www.ema.europa.eu/en/medicines/human/orphan-designations/eu-3-16-1684